EMA orphan designation EU/3/16/1717
Active substance: autologous mesenchymal stromal cells on a decellularised tracheal scaffold from a cadaveric donor
Designated 2016-08-29. Orphan designation status: Positive.
First published: 2016-10-03. Last updated: 2019-06-19.

Intended use: Treatment of tracheal stenosis

Source: https://www.ema.europa.eu/en/medicines/human/orphan-designations/eu-3-16-1717